EMA orphan designation EU/3/04/239
Active substance: Deuterium oxide
Designated 2004-10-20. Orphan designation status: Withdrawn.
First published: 2005-11-29. Last updated: 2005-11-29.

Intended use: Treatment of pancreatic cancer

Source: https://www.ema.europa.eu/en/medicines/human/orphan-designations/eu-3-04-239